EMA orphan designation EU/3/25/3138
Active substance: sutacimig
Designated 2025-10-20. Orphan designation status: Positive.
First published: 2026-04-14.

Intended use: Treatment of Glanzmann thrombasthenia

Source: https://www.ema.europa.eu/en/medicines/human/orphan-designations/eu-3-25-3138